EMA orphan designation EU/3/22/2605: Aucatzyl
Active substance: obecabtagene autoleucel
Designated 2022-04-13. Orphan designation status: Withdrawn. EMA product number: EMEA/H/C/005907.
First published: 2022-07-19. Last updated: 2025-07-17.

Intended use: Treatment of acute lymphoblastic leukaemia

Source: https://www.ema.europa.eu/en/medicines/human/orphan-designations/eu-3-22-2605